EMA orphan designation EU/3/10/808: Begedina
Active substance: murine monoclonal antibody against CD26
Designated 2010-11-26. Orphan designation status: Positive. EMA product number: EMEA/H/C/004144.
First published: 2010-12-15. Last updated: 2014-01-22.

Intended use: Treatment of graft-versus-host disease

Source: https://www.ema.europa.eu/en/medicines/human/orphan-designations/eu-3-10-808